EMA orphan designation EU/3/09/659
Active substance: tosedostat
Designated 2009-07-24. Orphan designation status: Withdrawn.
First published: 2009-09-11. Last updated: 2019-04-12.

Intended use: Treatment of acute myeloid leukaemia

Source: https://www.ema.europa.eu/en/medicines/human/orphan-designations/eu-3-09-659